EMA orphan designation EU/3/22/2635
Active substance: tamoxifen citrate
Orphan designation status: Positive.
First published: 2022-09-22. Last updated: 2022-11-07.

Intended use: Treatment of neuronal ceroid lipofuscinosis

Source: https://www.ema.europa.eu/en/medicines/human/orphan-designations/eu-3-22-2635